EMA orphan designation EU/3/17/1855: Epidyolex
Active substance: cannabidiol
Designated 2017-03-20. Orphan designation status: Positive. EMA product number: EMEA/H/C/004675.
First published: 2017-05-05. Last updated: 2023-06-14.

Intended use: Treatment of Lennox-Gastaut syndrome

Source: https://www.ema.europa.eu/en/medicines/human/orphan-designations/eu-3-17-1855